EMA orphan designation EU/3/25/3143
Active substance: ceruloplasmin
Designated 2025-10-20. Orphan designation status: Positive.
First published: 2026-04-14.

Intended use: Treatment of congenital aceruloplasminemia

Source: https://www.ema.europa.eu/en/medicines/human/orphan-designations/eu-3-25-3143